EMA orphan designation EU/3/15/1499
Active substance: adult human bone-marrow-derived, ex-vivo-expanded, pooled allogeneic mesenchymal stromal cells
Designated 2015-05-21. Orphan designation status: Positive.
First published: 2015-06-30. Last updated: 2021-10-13.

Intended use: Treatment of thromboangiitis obliterans (Buerger's disease)

Source: https://www.ema.europa.eu/en/medicines/human/orphan-designations/eu-3-15-1499